EMA orphan designation EU/3/14/1380
Active substance: ataluren
Designated 2014-11-19. Orphan designation status: Withdrawn.
First published: 2015-01-16. Last updated: 2018-02-22.

Intended use: Treatment of mucopolysaccharidosis type I

Source: https://www.ema.europa.eu/en/medicines/human/orphan-designations/eu-3-14-1380